EMA orphan designation EU/3/21/2447
Active substance: adeno-associated virus serotype 9 expressing human CLN5
Designated 2021-06-21. Orphan designation status: Positive.
First published: 2022-05-11.

Intended use: Treatment of neuronal ceroid lipofuscinosis

Source: https://www.ema.europa.eu/en/medicines/human/orphan-designations/eu-3-21-2447